EMA orphan designation EU/3/00/012
Active substance: nitisinone
Designated 2000-12-30. Orphan designation status: Expired.
First published: 2009-03-03. Last updated: 2015-05-08.

Intended use: Treatment of tyrosinaemia type 1

Source: https://www.ema.europa.eu/en/medicines/human/orphan-designations/eu-3-00-012